EMA orphan designation EU/3/13/1225
Active substance: Lonafarnib
Designated 2014-01-16. Orphan designation status: Positive.
First published: 2014-03-21. Last updated: 2019-08-19.

Intended use: Treatment of hepatitis delta virus infection

Source: https://www.ema.europa.eu/en/medicines/human/orphan-designations/eu-3-13-1225